EMA orphan designation EU/3/08/560
Designated 2008-09-05. Orphan designation status: Positive.
First published: 2009-04-24. Last updated: 2013-11-28.

Intended use: Treatment of moderate and severe closed traumatic brain injury

Source: https://www.ema.europa.eu/en/medicines/human/orphan-designations/eu-3-08-560